EMA orphan designation EU/3/19/2185
Active substance: Parsaclisib
Designated 2019-07-25. Orphan designation status: Withdrawn.
First published: 2019-09-24. Last updated: 2022-12-13.

Intended use: Treatment of marginal zone lymphoma

Source: https://www.ema.europa.eu/en/medicines/human/orphan-designations/eu-3-19-2185